EMA orphan designation EU/3/05/272
Active substance: histamine dihydrochloride
Designated 2005-04-11. Orphan designation status: Expired.
First published: 2007-03-07. Last updated: 2019-02-20.

Intended use: Treatment of acute myeloid leukaemia

Source: https://www.ema.europa.eu/en/medicines/human/orphan-designations/eu-3-05-272